EMA orphan designation EU/3/21/2485
Active substance: Humanised IgG1 monoclonal antibody against TfR1 conjugated to double stranded siRNA oligonucleotide against DMPK via a non-cleavable linker
Designated 2021-08-20. Orphan designation status: Positive.
First published: 2023-01-09.

Intended use: Treatment of myotonic disorders

Source: https://www.ema.europa.eu/en/medicines/human/orphan-designations/eu-3-21-2485